EMA orphan designation EU/3/23/2805
Active substance: ulviprubart
Designated 2023-07-25. Orphan designation status: Positive.
First published: 2024-01-30.

Intended use: Treatment of inclusion body myositis

Source: https://www.ema.europa.eu/en/medicines/human/orphan-designations/eu-3-23-2805